EMA orphan designation EU/3/13/1159
Active substance: Idelalisib
Designated 2013-07-17. Orphan designation status: Withdrawn.
First published: 2013-08-22. Last updated: 2013-11-15.

Intended use: Treatment of follicular lymphoma

Source: https://www.ema.europa.eu/en/medicines/human/orphan-designations/eu-3-13-1159